EMA orphan designation EU/3/23/2761
Active substance: bitopertin
Designated 2023-02-15. Orphan designation status: Positive.
First published: 2024-01-19.

Intended use: Treatment of erythropoietic protoporphyria

Source: https://www.ema.europa.eu/en/medicines/human/orphan-designations/eu-3-23-2761